EMA orphan designation EU/3/19/2206
Active substance: 2'-O-(2-methoxyethyl)-D-ribose antisense oligonucleotide targeting glial fibrillary acidic protein messenger ribonucleic acid
Designated 2019-10-17. Orphan designation status: Positive.
First published: 2020-01-21.

Intended use: Treatment of Alexander disease

Source: https://www.ema.europa.eu/en/medicines/human/orphan-designations/eu-3-19-2206